EMA orphan designation EU/3/25/3114
Active substance: RNA editing antisense oligonucleotide against the Z mutation of the human SERPINA1 mRNA transcript, sodium salt
Designated 2025-08-22. Orphan designation status: Withdrawn.
First published: 2026-04-10. Last updated: 2026-05-18.

Intended use: Treatment of alpha-1 antitrypsin deficiency

Source: https://www.ema.europa.eu/en/medicines/human/orphan-designations/eu-3-25-3114